EMA orphan designation EU/3/18/2076: Maapliv
Active substance: glycine; L-alanine; L-arginine; L-aspartic acid; L-cysteine; L-glutamic acid; L-histidine; L-lysine monohydrate; L-methionine; L-phenylalanine; L-proline; L-serine; L-threonine; L-tryptophan; L-tyrosine; taurine
Designated 2018-10-26. Orphan designation status: Positive. EMA product number: EMEA/H/C/005557.
First published: 2019-01-10. Last updated: 2020-02-03.

Intended use: Treatment of maple syrup urine disease

Source: https://www.ema.europa.eu/en/medicines/human/orphan-designations/eu-3-18-2076